EMA orphan designation EU/3/24/3019
Active substance: rilzabrutinib
Designated 2025-01-16. Orphan designation status: Positive.
First published: 2025-02-20.

Intended use: Treatment of autoimmune haemolytic anaemia

Source: https://www.ema.europa.eu/en/medicines/human/orphan-designations/eu-3-24-3019